EMA orphan designation EU/3/17/1968
Active substance: pyrazolo[1,5-a]pyrimidine, 3-[4-chloro-2-(4-morpholinyl)-5-thiazolyl]-7-(1-ethylpropyl)-2,5-dimethyl-pyrazolo[1,3-a]pyrimidine
Designated 2018-01-17. Orphan designation status: Positive.
First published: 2018-03-12. Last updated: 2018-03-12.

Intended use: Treatment of congenital adrenal hyperplasia

Source: https://www.ema.europa.eu/en/medicines/human/orphan-designations/eu-3-17-1968